EMA orphan designation EU/3/22/2597
Active substance: norucholic acid
Designated 2022-04-13. Orphan designation status: Positive.
First published: 2022-07-19.

Intended use: Treatment of primary biliary cholangitis

Source: https://www.ema.europa.eu/en/medicines/human/orphan-designations/eu-3-22-2597